EMA orphan designation EU/3/22/2717
Active substance: tricaprilin
Designated 2022-11-11. Orphan designation status: Positive.
First published: 2023-01-23.

Intended use: Treatment of West syndrome

Source: https://www.ema.europa.eu/en/medicines/human/orphan-designations/eu-3-22-2717